EMA orphan designation EU/3/16/1621
Active substance: Delta-9-tetrahydrocannabinol and cannabidiol from extracts of the Cannabis sativa L. plant
Designated 2016-02-17. Orphan designation status: Positive.
First published: 2016-04-07. Last updated: 2023-06-15.

Intended use: Treatment of glioma

Source: https://www.ema.europa.eu/en/medicines/human/orphan-designations/eu-3-16-1621